EMA orphan designation EU/3/22/2703
Active substance: Etidronate disodium
Designated 2022-10-11. Orphan designation status: Positive.
First published: 2023-01-24.

Intended use: Treatment of pseudoxanthoma elasticum

Source: https://www.ema.europa.eu/en/medicines/human/orphan-designations/eu-3-22-2703